EMA orphan designation EU/3/16/1728
Active substance: recombinant humanised monoclonal antibody against human complement component C5a
Designated 2016-08-29. Orphan designation status: Withdrawn.
First published: 2016-10-03. Last updated: 2017-10-19.

Intended use: Treatment of graft-versus-host disease

Source: https://www.ema.europa.eu/en/medicines/human/orphan-designations/eu-3-16-1728